EMA orphan designation EU/3/25/3030
Active substance: hydroxocobalamin acetate
Designated 2025-02-26. Orphan designation status: Positive.
First published: 2025-03-11.

Intended use: Treatment of homocystinuria and/or methylmalonic acidaemia due to genetic defects of intracellular cobalamin processing

Source: https://www.ema.europa.eu/en/medicines/human/orphan-designations/eu-3-25-3030